EMA orphan designation EU/3/02/125
Active substance: monoclonal antibody to human interleukin-6
Designated 2002-12-18. Orphan designation status: Withdrawn.
First published: 2008-08-18. Last updated: 2010-02-22.

Intended use: Treatment of post-transplantation lymphoproliferative disorders

Source: https://www.ema.europa.eu/en/medicines/human/orphan-designations/eu-3-02-125